EMA orphan designation EU/3/16/1761
Active substance: self-complementary adeno-associated viral vector serotype 9 containing the SGSH gene
Designated 2016-09-20. Orphan designation status: Positive.
First published: 2016-11-15. Last updated: 2022-09-16.

Intended use: Treatment of mucopolysaccharidosis, type IIIA (Sanfilippo A syndrome)

Source: https://www.ema.europa.eu/en/medicines/human/orphan-designations/eu-3-16-1761